EMA orphan designation EU/3/14/1363
Active substance: 4-[[(1S,4S)-5-[[4-[4-(oxazol-2-yl)phenoxy]phenyl]methyl]-2,5-diazabicyclo[2.2.1]hept-2-yl]methyl]benzoic acid (acebilustat)
Designated 2014-11-19. Orphan designation status: Positive.
First published: 2015-01-15. Last updated: 2019-02-19.

Intended use: Treatment of cystic fibrosis

Source: https://www.ema.europa.eu/en/medicines/human/orphan-designations/eu-3-14-1363